EMA orphan designation EU/3/05/304
Active substance: imatinib mesilate
Designated 2005-08-26. Orphan designation status: Expired.
First published: 2007-02-28. Last updated: 2015-02-10.

Intended use: Treatment of acute lymphoblastic leukaemia

Source: https://www.ema.europa.eu/en/medicines/human/orphan-designations/eu-3-05-304